EMA orphan designation EU/3/11/922
Active substance: Human platelet antigen-1a immunoglobulin
Designated 2011-10-27. Orphan designation status: Withdrawn.
First published: 2011-11-23. Last updated: 2026-05-26.

Intended use: Prevention of fetal and neonatal alloimmune thrombocytopenia

Source: https://www.ema.europa.eu/en/medicines/human/orphan-designations/eu-3-11-922